EMA orphan designation EU/3/08/600
Active substance: human anti-intercellular adhesion molecule-1 monoclonal antibody
Designated 2009-01-20. Orphan designation status: Positive.
First published: 2009-06-15. Last updated: 2009-06-15.

Intended use: Treatment of multiple myeloma

Source: https://www.ema.europa.eu/en/medicines/human/orphan-designations/eu-3-08-600